EMA orphan designation EU/3/22/2594
Active substance: heterologous human adult liver-derived stem cells
Designated 2022-03-16. Orphan designation status: Positive.
First published: 2022-06-20.

Intended use: Treatment of argininosuccinic aciduria

Source: https://www.ema.europa.eu/en/medicines/human/orphan-designations/eu-3-22-2594